EMA orphan designation EU/3/23/2877
Active substance: apilimod dimesilate
Designated 2024-01-12. Orphan designation status: Withdrawn.
First published: 2024-04-11.

Intended use: Treatment of amyotrophic lateral sclerosis

Source: https://www.ema.europa.eu/en/medicines/human/orphan-designations/eu-3-23-2877